EMA orphan designation EU/3/24/2920
Active substance: allogeneic non-alloreactive T cells edited with mRNA to disrupt TRAC and CD52 genes and transduced with lentiviral vector expressing a chimeric antigen receptor against CD22 and RQR8 depletion mechanism
Designated 2024-05-24. Orphan designation status: Positive.
First published: 2024-08-22.

Intended use: Treatment of acute lymphoblastic leukaemia

Source: https://www.ema.europa.eu/en/medicines/human/orphan-designations/eu-3-24-2920